EMA orphan designation EU/3/20/2249
Active substance: adeno-associated virus serotype 8 containing the human RdCVF sequence and the human RdCVFL sequence
Designated 2020-02-28. Orphan designation status: Positive.
First published: 2020-05-05.

Intended use: Treatment of inherited retinal dystrophies

Source: https://www.ema.europa.eu/en/medicines/human/orphan-designations/eu-3-20-2249